EMA orphan designation EU/3/12/1024: Myalepta
Active substance: Metreleptin
Designated 2012-07-17. Orphan designation status: Positive. EMA product number: EMEA/H/C/004218.
First published: 2012-08-31. Last updated: 2024-10-22.

Intended use: Treatment of Lawrence syndrome

Source: https://www.ema.europa.eu/en/medicines/human/orphan-designations/eu-3-12-1024